EMA orphan designation EU/3/14/1278
Active substance: adeno-associated viral vector serotype 2 containing the human CHM gene encoding human Rab escort protein 1
Designated 2014-06-04. Orphan designation status: Positive.
First published: 2014-07-10. Last updated: 2019-04-24.

Intended use: Treatment of choroideraemia

Source: https://www.ema.europa.eu/en/medicines/human/orphan-designations/eu-3-14-1278